EMA orphan designation EU/3/22/2616
Active substance: adeno-associated virus serotype 8 expressing the human gamma-sarcoglycan gene
Designated 2022-05-16. Orphan designation status: Positive.
First published: 2022-12-14.

Intended use: Treatment of limb-girdle muscular dystrophy

Source: https://www.ema.europa.eu/en/medicines/human/orphan-designations/eu-3-22-2616